EMA orphan designation EU/3/22/2588
Active substance: treprostinil sodium
Designated 2022-03-16. Orphan designation status: Positive.
First published: 2022-06-16. Last updated: 2024-10-22.

Intended use: Treatment of idiopathic pulmonary fibrosis

Source: https://www.ema.europa.eu/en/medicines/human/orphan-designations/eu-3-22-2588